EMA orphan designation EU/3/12/997
Active substance: N-Hydroxy-4-(3-methyl-2-(S)-phenyl-butyrylamino) benzamide
Designated 2012-06-06. Orphan designation status: Positive.
First published: 2012-07-04. Last updated: 2022-10-12.

Intended use: Treatment of schwannoma

Source: https://www.ema.europa.eu/en/medicines/human/orphan-designations/eu-3-12-997